EMA orphan designation EU/3/05/326
Active substance: Peptide 144 TGF-beta1-inhibitor (TSLDASIIWAMMQN)
Designated 2005-10-28. Orphan designation status: Positive.
First published: 2007-05-30. Last updated: 2010-06-23.

Intended use: Treatment of systemic sclerosis

Source: https://www.ema.europa.eu/en/medicines/human/orphan-designations/eu-3-05-326